EMA orphan designation EU/3/20/2383
Active substance: perflubron
Designated 2020-12-09. Orphan designation status: Withdrawn.
First published: 2021-05-26. Last updated: 2026-05-13.

Intended use: Treatment of respiratory distress syndrome

Source: https://www.ema.europa.eu/en/medicines/human/orphan-designations/eu-3-20-2383